EMA orphan designation EU/3/10/796
Active substance: recombinant humanised anti-human interleukin-1 beta monoclonal antibody
Designated 2010-10-01. Orphan designation status: Withdrawn.
First published: 2010-10-14. Last updated: 2018-07-18.

Intended use: Treatment of Behçet's Disease

Source: https://www.ema.europa.eu/en/medicines/human/orphan-designations/eu-3-10-796